EMA orphan designation EU/3/05/288: Nurzigma
Active substance: 4-[3-(Methylsulfonyl)phenyl]-1-propylpiperidine x HC1 (pridopidine hydrochloride)
Designated 2005-06-20. Orphan designation status: Positive. EMA product number: EMEA/H/C/006261.
First published: 2007-07-04. Last updated: 2026-05-11.

Intended use: Treatment of Huntington’s disease

Source: https://www.ema.europa.eu/en/medicines/human/orphan-designations/eu-3-05-288